EMA orphan designation EU/3/07/491
Active substance: Interferon gamma
Designated 2007-10-29. Orphan designation status: Positive.
First published: 2008-07-02. Last updated: 2013-03-01.

Intended use: Treatment of idiopathic pulmonary fibrosis

Source: https://www.ema.europa.eu/en/medicines/human/orphan-designations/eu-3-07-491